EMA orphan designation EU/3/22/2584
Active substance: Hymecromone
Designated 2022-03-16. Orphan designation status: Positive.
First published: 2022-06-16.

Intended use: Treatment of spinal cord injury

Source: https://www.ema.europa.eu/en/medicines/human/orphan-designations/eu-3-22-2584